EMA orphan designation EU/3/08/534
Active substance: allogeneic human umbilical cord tissue-derived cells
Designated 2008-04-01. Orphan designation status: Withdrawn.
First published: 2008-07-10. Last updated: 2010-06-23.

Intended use: Treatment of retinitis pigmentosa

Source: https://www.ema.europa.eu/en/medicines/human/orphan-designations/eu-3-08-534